EMA orphan designation EU/3/19/2213: Skytrofa (previously Lonapegsomatropin Ascendis Pharma)
Active substance: lonapegsomatropin
Designated 2019-10-17. Orphan designation status: Positive. EMA product number: EMEA/H/C/005367.
First published: 2020-01-22. Last updated: 2022-02-17.

Intended use: Treatment of growth hormone deficiency

Source: https://www.ema.europa.eu/en/medicines/human/orphan-designations/eu-3-19-2213